EMA orphan designation EU/3/14/1242
Active substance: 3-Chloro-4-fluorophenyl-[4-fluoro-4-{[(5-methylpyrimidin-2-ylmethyl) amino]methyl}piperidin-1-yl]methanone
Designated 2014-02-19. Orphan designation status: Positive.
First published: 2014-04-02. Last updated: 2018-10-18.

Intended use: Treatment of Rett syndrome

Source: https://www.ema.europa.eu/en/medicines/human/orphan-designations/eu-3-14-1242